EMA orphan designation EU/3/16/1811
Active substance: 5-aminolevulinic acid
Designated 2017-01-12. Orphan designation status: Positive.
First published: 2017-07-13. Last updated: 2023-03-10.

Intended use: Treatment of glioma

Source: https://www.ema.europa.eu/en/medicines/human/orphan-designations/eu-3-16-1811